EMA orphan designation EU/3/12/955
Active substance: Doxycycline hyclate
Designated 2012-04-02. Orphan designation status: Positive.
First published: 2012-05-24. Last updated: 2012-05-24.

Intended use: Treatment of familial amyloid polyneuropathy

Source: https://www.ema.europa.eu/en/medicines/human/orphan-designations/eu-3-12-955